EMA orphan designation EU/3/05/309
Active substance: sodium valproate
Designated 2005-08-26. Orphan designation status: Withdrawn.
First published: 2005-11-29. Last updated: 2014-01-13.

Intended use: Treatment of 5q spinal muscular atrophy

Source: https://www.ema.europa.eu/en/medicines/human/orphan-designations/eu-3-05-309